EMA orphan designation EU/3/17/1943
Active substance: recombinant adeno-associated viral vector serotype 9 containing human iduronate-2-sulfatase gene
Designated 2017-10-12. Orphan designation status: Positive.
First published: 2018-01-15. Last updated: 2018-01-15.

Intended use: Treatment of mucopolysaccharidosis type II (Hunter's syndrome)

Source: https://www.ema.europa.eu/en/medicines/human/orphan-designations/eu-3-17-1943